EMA orphan designation EU/3/14/1269
Active substance: Lutetium (177Lu) edotreotide
Designated 2014-06-04. Orphan designation status: Positive.
First published: 2014-07-10. Last updated: 2017-09-05.

Intended use: Treatment of gastro-entero-pancreatic neuroendocrine tumours

Source: https://www.ema.europa.eu/en/medicines/human/orphan-designations/eu-3-14-1269